EMA orphan designation EU/3/16/1784
Active substance: particles comprised of methacrylic acid based co-polymer, cross-linked with a bi-functional cross-linker, purified to bind L-phenylalanine and L-phenylalanine containing peptides
Designated 2016-11-18. Orphan designation status: Positive.
First published: 2016-12-13. Last updated: 2016-12-13.

Intended use: Treatment of hyperphenylalaninaemia

Source: https://www.ema.europa.eu/en/medicines/human/orphan-designations/eu-3-16-1784